EMA orphan designation EU/3/18/2129
Active substance: miglustat
Designated 2019-01-11. Orphan designation status: Withdrawn.
First published: 2019-03-27. Last updated: 2023-03-24.

Intended use: Treatment of glycogen storage disease type II (Pompe's disease)

Source: https://www.ema.europa.eu/en/medicines/human/orphan-designations/eu-3-18-2129